EMA orphan designation EU/3/22/2573
Active substance: vatiquinone
Designated 2022-02-24. Orphan designation status: Withdrawn.
First published: 2022-04-28. Last updated: 2026-05-13.

Intended use: Treatment of mitochondrial encephalomyopathy, lactic acidosis, and stroke-like episodes

Source: https://www.ema.europa.eu/en/medicines/human/orphan-designations/eu-3-22-2573